EMA orphan designation EU/3/14/1316
Active substance: recombinant factor VIIa modified with three terminal repeats derived from the β chain of human chorionic gonadotropin
Designated 2014-08-22. Orphan designation status: Positive.
First published: 2014-10-01. Last updated: 2021-09-16.

Intended use: Treatment of haemophilia A

Source: https://www.ema.europa.eu/en/medicines/human/orphan-designations/eu-3-14-1316